EMA orphan designation EU/3/20/2375
Active substance: 2'-O-(2-methoxyethyl) phosphorothioate antisense oligonucleotide targeting CD49d RNA
Designated 2020-12-09. Orphan designation status: Positive.
First published: 2021-05-25.

Intended use: Treatment of Duchenne muscular dystrophy

Source: https://www.ema.europa.eu/en/medicines/human/orphan-designations/eu-3-20-2375